EMA orphan designation EU/3/12/984
Active substance: 1-[(3R)-3-[4-amino-3-(4-phenoxyphenyl)-1H-pyrazolo[3,4 d]pyrimidin-1-yl]-1-piperidinyl]-2-propen-1-one (ibrutinib)
Designated 2012-04-26. Orphan designation status: Withdrawn.
First published: 2012-06-18. Last updated: 2021-10-21.

Intended use: Treatment of chronic lymphocytic leukaemia

Source: https://www.ema.europa.eu/en/medicines/human/orphan-designations/eu-3-12-984